EMA orphan designation EU/3/08/609
Active substance: adeno-associated viral vector serotype 5 containing the human ABCA4 gene
Designated 2009-02-07. Orphan designation status: Withdrawn.
First published: 2009-10-30. Last updated: 2014-10-16.

Intended use: Treatment of Stargardt's disease

Source: https://www.ema.europa.eu/en/medicines/human/orphan-designations/eu-3-08-609